EMA orphan designation EU/3/21/2477
Active substance: Adeno-associated viral vector serotype Anc80 containing the 3' portion of human OTOF gene, adeno-associated viral vector serotype Anc80 containing the 5' portion of human OTOF gene
Designated 2021-07-19. Orphan designation status: Positive.
First published: 2021-11-16. Last updated: 2026-05-11.

Intended use: Treatment of otoferlin gene-mediated hearing loss

Source: https://www.ema.europa.eu/en/medicines/human/orphan-designations/eu-3-21-2477